EMA orphan designation EU/3/01/040
Active substance: Fomepizole
Designated 2001-05-30. Orphan designation status: Withdrawn.
First published: 2009-02-24. Last updated: 2011-05-06.

Intended use: Treatment of methanol poisoning

Source: https://www.ema.europa.eu/en/medicines/human/orphan-designations/eu-3-01-040